EMA orphan designation EU/3/17/1961: Xospata
Active substance: Gilteritinib
Designated 2018-01-17. Orphan designation status: Positive. EMA product number: EMEA/H/C/004752.
First published: 2018-03-13. Last updated: 2019-11-08.

Intended use: Treatment of acute myeloid leukaemia

Source: https://www.ema.europa.eu/en/medicines/human/orphan-designations/eu-3-17-1961